EMA orphan designation EU/3/01/073
Active substance: recombinant human monoclonal antibody to hsp90
Designated 2001-12-05. Orphan designation status: Withdrawn.
First published: 2009-03-12. Last updated: 2015-02-12.

Intended use: Treatment of invasive fungal infections

Source: https://www.ema.europa.eu/en/medicines/human/orphan-designations/eu-3-01-073